EMA orphan designation EU/3/14/1357
Active substance: imatinib
Designated 2014-11-19. Orphan designation status: Positive.
First published: 2015-01-15. Last updated: 2021-08-06.

Intended use: Treatment of acute respiratory distress syndrome (ARDS)

Source: https://www.ema.europa.eu/en/medicines/human/orphan-designations/eu-3-14-1357